EMA orphan designation EU/3/23/2754
Designated 2023-02-15. Orphan designation status: Withdrawn.
First published: 2024-01-24. Last updated: 2025-01-15.

Intended use: Treatment of Huntington's disease

Source: https://www.ema.europa.eu/en/medicines/human/orphan-designations/eu-3-23-2754